EMA orphan designation EU/3/21/2412
Active substance: Adeno-associated virus serotype 5 containing the human NR2E3 gene
Designated 2021-02-19. Orphan designation status: Positive.
First published: 2022-02-24.

Intended use: Treatment of Leber's congenital amaurosis

Source: https://www.ema.europa.eu/en/medicines/human/orphan-designations/eu-3-21-2412